EMA orphan designation EU/3/22/2687
Active substance: clarithromycin; clofazimine; rifabutin
Designated 2022-08-10. Orphan designation status: Positive.
First published: 2022-12-15.

Intended use: Treatment of nontuberculous mycobacterial lung disease

Source: https://www.ema.europa.eu/en/medicines/human/orphan-designations/eu-3-22-2687